EMA orphan designation EU/3/07/483
Active substance: amonafide L-malate
Designated 2007-10-22. Orphan designation status: Withdrawn.
First published: 2009-09-17. Last updated: 2011-05-18.

Intended use: Treatment of acute myeloid leukaemia

Source: https://www.ema.europa.eu/en/medicines/human/orphan-designations/eu-3-07-483